EMA orphan designation EU/3/12/983
Active substance: heterologous human adult liver-derived stem cells
Designated 2012-04-26. Orphan designation status: Positive.
First published: 2012-06-18. Last updated: 2021-08-26.

Intended use: Treatment of acute liver failure

Source: https://www.ema.europa.eu/en/medicines/human/orphan-designations/eu-3-12-983